EMA orphan designation EU/3/17/1927
Active substance: pracinostat
Designated 2017-10-16. Orphan designation status: Withdrawn.
First published: 2018-01-09. Last updated: 2022-02-18.

Intended use: Treatment of acute myeloid leukaemia

Source: https://www.ema.europa.eu/en/medicines/human/orphan-designations/eu-3-17-1927